EMA orphan designation EU/3/21/2566
Active substance: adeno-associated virus serotype 9 expressing the human fukutin related protein and target sequence of the miR-208a
Designated 2022-01-14. Orphan designation status: Positive.
First published: 2022-06-09.

Intended use: Treatment of limb girdle muscular dystrophy

Source: https://www.ema.europa.eu/en/medicines/human/orphan-designations/eu-3-21-2566